EMA orphan designation EU/3/16/1773
Active substance: allogenic cytomegalovirus-specific cytotoxic T lymphocytes (adimlecleucel)
Designated 2016-11-18. Orphan designation status: Withdrawn.
First published: 2016-12-13. Last updated: 2022-09-16.

Intended use: Treatment of cytomegalovirus infection in patients with impaired cell-mediated immunity

Source: https://www.ema.europa.eu/en/medicines/human/orphan-designations/eu-3-16-1773